EMA orphan designation EU/3/15/1487
Active substance: reduced oxidised N-acetyl heparin (roneparstat)
Designated 2015-05-21. Orphan designation status: Withdrawn.
First published: 2015-06-30. Last updated: 2021-09-02.

Intended use: Treatment of plasma cell myeloma

Source: https://www.ema.europa.eu/en/medicines/human/orphan-designations/eu-3-15-1487